EMA orphan designation EU/3/14/1390: Orblid
Active substance: bevacizumab
Designated 2014-12-16. Orphan designation status: Positive. EMA product number: EMEA/H/C/006392.
First published: 2015-02-26. Last updated: 2026-05-22.

Intended use: Treatment of hereditary haemorrhagic telangiectasia

Source: https://www.ema.europa.eu/en/medicines/human/orphan-designations/eu-3-14-1390